EMA orphan designation EU/3/21/2451
Active substance: Bomedemstat ditosilate
Designated 2021-06-21. Orphan designation status: Positive.
First published: 2022-05-11. Last updated: 2023-05-02.

Intended use: Treatment of essential thrombocythaemia

Source: https://www.ema.europa.eu/en/medicines/human/orphan-designations/eu-3-21-2451